EMA orphan designation EU/3/15/1578
Active substance: adenovirus associated viral vector serotype 8 containing the human CNGB3 gene
Designated 2015-11-11. Orphan designation status: Positive.
First published: 2016-01-22. Last updated: 2024-10-22.

Intended use: Treatment of achromatopsia caused by mutations in the CNGB3 gene

Source: https://www.ema.europa.eu/en/medicines/human/orphan-designations/eu-3-15-1578